EMA orphan designation EU/3/25/3086
Active substance: ribavirin
Designated 2025-07-18. Orphan designation status: Positive.
First published: 2025-09-04.

Intended use: Treatment of hepatitis E

Source: https://www.ema.europa.eu/en/medicines/human/orphan-designations/eu-3-25-3086